EMA orphan designation EU/3/02/088
Active substance: Colistimethate sodium
Designated 2002-02-19. Orphan designation status: Withdrawn.
First published: 2003-01-08. Last updated: 2011-11-18.

Intended use: Treatment of Pseudomonas aeruginosa lung infection (including colonisation) in cystic fibrosis

Source: https://www.ema.europa.eu/en/medicines/human/orphan-designations/eu-3-02-088